EMA orphan designation EU/3/16/1740
Active substance: adeno-associated viral vector serotype 2/2 containing a gene encoding the channelrhodopsin-2 protein
Designated 2016-10-14. Orphan designation status: Withdrawn.
First published: 2016-11-15. Last updated: 2021-06-11.

Intended use: Treatment of retinitis pigmentosa

Source: https://www.ema.europa.eu/en/medicines/human/orphan-designations/eu-3-16-1740